EMA orphan designation EU/3/03/148
Active substance: chimeric anti-interleukin-6 monoclonal antibody
Designated 2003-06-30. Orphan designation status: Withdrawn.
First published: 2003-07-08. Last updated: 2011-11-11.

Intended use: Treatment of renal-cell carcinoma

Source: https://www.ema.europa.eu/en/medicines/human/orphan-designations/eu-3-03-148